EMA orphan designation EU/3/16/1803
Active substance: Metformin
Designated 2016-12-12. Orphan designation status: Positive.
First published: 2017-01-09. Last updated: 2022-11-11.

Intended use: Treatment of progressive myoclonic epilepsy type 2 (Lafora disease)

Source: https://www.ema.europa.eu/en/medicines/human/orphan-designations/eu-3-16-1803